EMA orphan designation EU/3/16/1694
Active substance: autologous CD4+ and CD8+ T cells transduced with lentiviral vector containing an affinity-enhanced T-cell receptor targeting the New York esophageal antigen-1
Designated 2016-07-14. Orphan designation status: Positive.
First published: 2016-09-06. Last updated: 2024-10-11.

Intended use: Treatment of soft tissue sarcoma

Source: https://www.ema.europa.eu/en/medicines/human/orphan-designations/eu-3-16-1694